EMA orphan designation EU/3/12/996
Active substance: N-Hydroxy-4-(3-methyl-2-(S)-phenyl-butyrylamino) benzamide
Designated 2012-06-06. Orphan designation status: Positive.
First published: 2012-07-04. Last updated: 2022-10-12.

Intended use: Treatment of meningioma

Source: https://www.ema.europa.eu/en/medicines/human/orphan-designations/eu-3-12-996